EMA orphan designation EU/3/21/2442
Active substance: adeno-associated virus serotype 9 expressing the cDNA for human MECP2
Designated 2021-05-20. Orphan designation status: Withdrawn.
First published: 2022-03-18. Last updated: 2022-03-30.

Intended use: Treatment of Rett syndrome

Source: https://www.ema.europa.eu/en/medicines/human/orphan-designations/eu-3-21-2442